EMA orphan designation EU/3/04/211
Active substance: defibrotide
Designated 2004-07-29. Orphan designation status: Withdrawn.
First published: 2005-12-12. Last updated: 2025-06-05.

Intended use: Prevention of hepatic veno-occlusive disease

Source: https://www.ema.europa.eu/en/medicines/human/orphan-designations/eu-3-04-211